EMA orphan designation EU/3/24/2988
Active substance: 7-ethyl-10-hydroxy-camptothecin
Designated 2024-11-11. Orphan designation status: Positive.
First published: 2024-11-19.

Intended use: Treatment of glioma

Source: https://www.ema.europa.eu/en/medicines/human/orphan-designations/eu-3-24-2988